EMA orphan designation EU/3/25/3133
Active substance: adeno-associated viral vector serotype ShH10 containing a codon-optimised human NDP gene
Orphan designation status: Positive.
First published: 2026-04-10.

Intended use: Treatment of NDP gene-related disorders

Source: https://www.ema.europa.eu/en/medicines/human/orphan-designations/eu-3-25-3133